EMA orphan designation EU/3/20/2308
Active substance: allogeneic umbilical cord tissue-derived mesenchymal stromal cells ex vivo expanded
Designated 2020-08-21. Orphan designation status: Positive.
First published: 2020-11-24.

Intended use: Prevention of bronchopulmonary dysplasia

Source: https://www.ema.europa.eu/en/medicines/human/orphan-designations/eu-3-20-2308